EMA orphan designation EU/3/25/3099
Active substance: telitacicept
Designated 2025-07-18. Orphan designation status: Positive.
First published: 2025-09-04.

Intended use: Treatment of myasthenia gravis

Source: https://www.ema.europa.eu/en/medicines/human/orphan-designations/eu-3-25-3099